EMA orphan designation EU/3/25/3084
Active substance: palmitoyl-conjugated tricyclo-DNA antisense oligonucleotide 5'-Palm-C6-*GGA GAT GgC AGT TTC-3
Designated 2025-07-18. Orphan designation status: Positive.
First published: 2025-09-04.

Intended use: Treatment of Duchenne muscular dystrophy

Source: https://www.ema.europa.eu/en/medicines/human/orphan-designations/eu-3-25-3084